EMA orphan designation EU/3/22/2707
Active substance: Eculizumab
Designated 2022-10-11. Orphan designation status: Positive.
First published: 2023-01-24.

Intended use: Treatment of Guillain-Barré syndrome

Source: https://www.ema.europa.eu/en/medicines/human/orphan-designations/eu-3-22-2707